EMA orphan designation EU/3/03/132
Active substance: Caffeine citrate
Designated 2003-02-18. Orphan designation status: Expired.
First published: 2007-03-07. Last updated: 2019-07-11.

Intended use: Treatment of primary apnoea of premature newborns

Source: https://www.ema.europa.eu/en/medicines/human/orphan-designations/eu-3-03-132